EMA orphan designation EU/3/15/1460
Active substance: lenvatinib
Designated 2015-03-19. Orphan designation status: Withdrawn.
First published: 2015-05-11. Last updated: 2018-10-22.

Intended use: Treatment of hepatocellular carcinoma

Source: https://www.ema.europa.eu/en/medicines/human/orphan-designations/eu-3-15-1460